EMA orphan designation EU/3/01/050
Active substance: zinc acetate dihydrate
Designated 2001-07-31. Orphan designation status: Expired.
First published: 2009-03-03. Last updated: 2015-03-06.

Intended use: Treatment of Wilson's disease

Source: https://www.ema.europa.eu/en/medicines/human/orphan-designations/eu-3-01-050